EMA orphan designation EU/3/22/2669
Active substance: toripalimab
Designated 2022-07-18. Orphan designation status: Withdrawn.
First published: 2023-01-23.

Intended use: Treatment of nasopharyngeal cancer

Source: https://www.ema.europa.eu/en/medicines/human/orphan-designations/eu-3-22-2669